EMA orphan designation EU/3/21/2551
Active substance: adeno-associated virus vector serotype 9 encoding the human GRN gene
Designated 2021-12-10. Orphan designation status: Positive.
First published: 2022-05-03. Last updated: 2022-05-03.

Intended use: Treatment of frontotemporal dementia

Source: https://www.ema.europa.eu/en/medicines/human/orphan-designations/eu-3-21-2551